EMA orphan designation EU/3/14/1379
Active substance: Dantrolene sodium
Designated 2014-11-19. Orphan designation status: Positive.
First published: 2015-01-16. Last updated: 2015-01-16.

Intended use: Treatment of malignant hyperthermia

Source: https://www.ema.europa.eu/en/medicines/human/orphan-designations/eu-3-14-1379